EMA orphan designation EU/3/18/2093
Active substance: human apotransferrin
Designated 2018-11-19. Orphan designation status: Positive.
First published: 2019-02-19.

Intended use: Treatment of beta thalassaemia intermedia and major

Source: https://www.ema.europa.eu/en/medicines/human/orphan-designations/eu-3-18-2093